EMA orphan designation EU/3/09/686
Active substance: NGR-human tumour necrosis factor
Designated 2009-11-09. Orphan designation status: Positive.
First published: 2009-11-18. Last updated: 2009-11-18.

Intended use: Treatment of hepatocellular carcinoma

Source: https://www.ema.europa.eu/en/medicines/human/orphan-designations/eu-3-09-686